EMA orphan designation EU/3/18/2115
Active substance: human anti-promyostatin monoclonal antibody (apitegromab)
Designated 2018-12-14. Orphan designation status: Positive.
First published: 2019-03-07. Last updated: 2025-02-24.

Intended use: Treatment of spinal muscular atrophy

Source: https://www.ema.europa.eu/en/medicines/human/orphan-designations/eu-3-18-2115